EMA orphan designation EU/3/20/2328
Active substance: human frataxin fused to TAT cell-penetrating peptide
Designated 2020-08-21. Orphan designation status: Positive.
First published: 2020-11-25.

Intended use: Treatment of Friedreich’s ataxia

Source: https://www.ema.europa.eu/en/medicines/human/orphan-designations/eu-3-20-2328